EMA orphan designation EU/3/16/1795
Active substance: adeno-associated viral vector serotype 8 containing the human CNGA3 gene under the control of a cone arrestin promoter
Orphan designation status: Positive.
First published: 2016-01-09. Last updated: 2016-01-09.

Intended use: Treatment of achromatopsia caused by mutations in the CNGA3 gene

Source: https://www.ema.europa.eu/en/medicines/human/orphan-designations/eu-3-16-1795